EMA orphan designation EU/3/02/120
Active substance: Duramycin
Designated 2002-11-13. Orphan designation status: Positive.
First published: 2005-12-12. Last updated: 2022-02-04.

Intended use: Treatment of cystic fibrosis

Source: https://www.ema.europa.eu/en/medicines/human/orphan-designations/eu-3-02-120